EMA orphan designation EU/3/07/519: Livtencity
Active substance: Maribavir
Designated 2007-12-18. Orphan designation status: Positive. EMA product number: EMEA/H/C/005787.
First published: 2009-09-23. Last updated: 2022-11-24.

Intended use: Prevention of cytomegalovirus disease in patients with impaired cell-mediated immunity

Source: https://www.ema.europa.eu/en/medicines/human/orphan-designations/eu-3-07-519